EMA orphan designation EU/3/14/1261: Blectifor
Active substance: Caffeine citrate
Designated 2014-04-11. Orphan designation status: Positive. EMA product number: EMEA/H/C/004100.
First published: 2014-06-05. Last updated: 2014-06-05.

Intended use: Prevention of bronchopulmonary dysplasia

Source: https://www.ema.europa.eu/en/medicines/human/orphan-designations/eu-3-14-1261